EMA orphan designation EU/3/22/2691
Active substance: amphotericin B
Designated 2022-08-10. Orphan designation status: Withdrawn.
First published: 2022-12-15.

Intended use: Treatment of cryptococcosis

Source: https://www.ema.europa.eu/en/medicines/human/orphan-designations/eu-3-22-2691